EMA orphan designation EU/3/10/831
Active substance: autologous tumour-derived immunoglobulin idiotype coupled to keyhole limpet hemocyanin
Designated 2011-02-23. Orphan designation status: Positive.
First published: 2011-03-04. Last updated: 2011-03-04.

Intended use: Treatment of mantle cell lymphoma

Source: https://www.ema.europa.eu/en/medicines/human/orphan-designations/eu-3-10-831